EMA orphan designation EU/3/13/1101
Active substance: progesterone
Designated 2013-02-08. Orphan designation status: Withdrawn.
First published: 2013-03-04. Last updated: 2014-10-16.

Intended use: Treatment of moderate and severe traumatic brain injury

Source: https://www.ema.europa.eu/en/medicines/human/orphan-designations/eu-3-13-1101